EMA orphan designation EU/3/09/667
Active substance: expanded human allogeneic mesenchymal adult stem cells extracted from adipose tissue (darvadstrocel)
Designated 2009-10-08. Orphan designation status: Withdrawn.
First published: 2009-10-20. Last updated: 2025-04-24.

Intended use: Treatment of anal fistula

Source: https://www.ema.europa.eu/en/medicines/human/orphan-designations/eu-3-09-667